EMA orphan designation EU/3/14/1420
Active substance: emtricitabine
Designated 2015-01-15. Orphan designation status: Positive.
First published: 2015-03-06. Last updated: 2015-03-06.

Intended use: Treatment of Aicardi-Goutières syndrome

Source: https://www.ema.europa.eu/en/medicines/human/orphan-designations/eu-3-14-1420